EMA orphan designation EU/3/05/290
Active substance: Humanised antibody fragment (EP-CAM)-truncated Pseudomonas exotoxin A fusion protein
Designated 2005-06-20. Orphan designation status: Positive.
First published: 2005-12-12. Last updated: 2005-12-12.

Intended use: Treatment of Ep-CAM-YES squamous cell carcinoma of the head and neck

Source: https://www.ema.europa.eu/en/medicines/human/orphan-designations/eu-3-05-290